EMA orphan designation EU/3/07/515
Active substance: tegafur; gimeracil; oteracil potassium
Designated 2007-12-20. Orphan designation status: Withdrawn.
First published: 2009-09-23. Last updated: 2011-03-28.

Intended use: Treatment of gastric cancer

Source: https://www.ema.europa.eu/en/medicines/human/orphan-designations/eu-3-07-515